EMA orphan designation EU/3/20/2348
Active substance: poly(oxy-1,2-ethanediyl), alpha-(carboxymethyl)-omega-methoxy-, amide with cystathionine γ-lyase [Pyridoxal 5’-phosphate cofactor] (synthetic engineered human), tetramer
Designated 2020-10-19. Orphan designation status: Positive.
First published: 2021-02-18.

Intended use: Treatment of homocystinuria

Source: https://www.ema.europa.eu/en/medicines/human/orphan-designations/eu-3-20-2348